EMA orphan designation EU/3/11/893
Active substance: cardiotrophin-1
Designated 2011-08-30. Orphan designation status: Positive.
First published: 2011-09-21. Last updated: 2011-09-21.

Intended use: Treatment of acute liver failure

Source: https://www.ema.europa.eu/en/medicines/human/orphan-designations/eu-3-11-893